EMA orphan designation EU/3/20/2369: Winrevair
Active substance: sotatercept
Designated 2020-12-09. Orphan designation status: Positive. EMA product number: EMEA/H/C/005647.
First published: 2021-05-21. Last updated: 2024-06-28.

Intended use: Treatment of pulmonary arterial hypertension

Source: https://www.ema.europa.eu/en/medicines/human/orphan-designations/eu-3-20-2369